EMA orphan designation EU/3/21/2457
Active substance: adeno-associated viral vector LK03 encoding human methylmalonyl-CoA mutase
Designated 2021-06-21. Orphan designation status: Positive.
First published: 2022-05-11.

Intended use: Treatment of methylmalonic acidaemia

Source: https://www.ema.europa.eu/en/medicines/human/orphan-designations/eu-3-21-2457